EMA orphan designation EU/3/16/1697
Active substance: brincidofovir
Designated 2016-07-14. Orphan designation status: Positive.
First published: 2016-09-06. Last updated: 2024-10-11.

Intended use: Treatment of adenovirus infection in immunocompromised patients

Source: https://www.ema.europa.eu/en/medicines/human/orphan-designations/eu-3-16-1697